EMA orphan designation EU/3/12/1022: Myalepta
Active substance: Metreleptin
Designated 2012-07-17. Orphan designation status: Positive. EMA product number: EMEA/H/C/004218.
First published: 2012-08-31. Last updated: 2024-10-22.

Intended use: Treatment of familial partial lipodystrophy

Source: https://www.ema.europa.eu/en/medicines/human/orphan-designations/eu-3-12-1022